EMA orphan designation EU/3/10/737: Ravicti
Active substance: Glyceryl tri-(4-phenylbutyrate)
Designated 2010-06-10. Orphan designation status: Positive. EMA product number: EMEA/H/C/003822.
First published: 2010-06-24. Last updated: 2022-11-18.

Intended use: Treatment of hyperargininaemia

Source: https://www.ema.europa.eu/en/medicines/human/orphan-designations/eu-3-10-737